EMA orphan designation EU/3/24/2945
Active substance: emavusertib
Designated 2024-07-25. Orphan designation status: Positive.
First published: 2024-09-13.

Intended use: Treatment of primary large B-cell lymphoma of immune-privileged sites

Source: https://www.ema.europa.eu/en/medicines/human/orphan-designations/eu-3-24-2945